EMA orphan designation EU/3/25/3035: Palsonify
Active substance: paltusotine
Designated 2025-02-26. Orphan designation status: Positive. EMA product number: EMEA/H/C/006636.
First published: 2025-03-11.

Intended use: Treatment of acromegaly

Source: https://www.ema.europa.eu/en/medicines/human/orphan-designations/eu-3-25-3035